EMA orphan designation EU/3/12/998: Waskyra
Active substance: autologous CD34+ cells transfected with lentiviral vector containing the Wiskott-Aldrich syndrome protein gene (etuvetidigene autotemcel)
Designated 2012-06-06. Orphan designation status: Positive. EMA product number: EMEA/H/C/006525.
First published: 2012-07-04. Last updated: 2023-03-27.

Intended use: Treatment of Wiskott-Aldrich syndrome

Source: https://www.ema.europa.eu/en/medicines/human/orphan-designations/eu-3-12-998